EMA orphan designation EU/3/03/183
Active substance: temocillin sodium
Designated 2004-01-14. Orphan designation status: Positive.
First published: 2004-09-23. Last updated: 2014-07-14.

Intended use: Treatment of Burkholderia cepacia lung infection in cystic fibrosis

Source: https://www.ema.europa.eu/en/medicines/human/orphan-designations/eu-3-03-183